EMA orphan designation EU/3/06/413
Active substance: Budesonide
Designated 2006-11-03. Orphan designation status: Positive.
First published: 2009-02-20. Last updated: 2011-06-21.

Intended use: Treatment of graft-versus-host disease

Source: https://www.ema.europa.eu/en/medicines/human/orphan-designations/eu-3-06-413